EMA orphan designation EU/3/25/3146
Active substance: autologous peripheral blood-derived CD34+ haematopoietic stem and progenitor cells transduced with a lentiviral vector containing the murine Glb1 gene
Designated 2025-10-22. Orphan designation status: Positive.
First published: 2026-04-10.

Intended use: Treatment of Glb1-related disorders

Source: https://www.ema.europa.eu/en/medicines/human/orphan-designations/eu-3-25-3146